EMA orphan designation EU/3/20/2391
Active substance: idursulfase
Designated 2021-01-06. Orphan designation status: Withdrawn.
First published: 2021-10-18. Last updated: 2023-01-27.

Intended use: Treatment of mucopolysaccharidosis type II (Hunter's syndrome)

Source: https://www.ema.europa.eu/en/medicines/human/orphan-designations/eu-3-20-2391